EMA orphan designation EU/3/16/1666
Active substance: rimiducid
Designated 2016-05-30. Orphan designation status: Withdrawn.
First published: 2016-06-23. Last updated: 2022-04-07.

Intended use: Treatment of graft-versus-host disease

Source: https://www.ema.europa.eu/en/medicines/human/orphan-designations/eu-3-16-1666